EMA orphan designation EU/3/09/707
Active substance: macitentan
Designated 2010-01-28. Orphan designation status: Withdrawn.
First published: 2010-02-25. Last updated: 2026-05-26.

Intended use: Treatment of idiopathic pulmonary fibrosis

Source: https://www.ema.europa.eu/en/medicines/human/orphan-designations/eu-3-09-707